EMA orphan designation EU/3/17/1953
Active substance: pegunigalsidase alfa
Designated 2017-12-12. Orphan designation status: Withdrawn.
First published: 2018-01-23. Last updated: 2023-05-08.

Intended use: Treatment of Fabry disease

Source: https://www.ema.europa.eu/en/medicines/human/orphan-designations/eu-3-17-1953